EMA orphan designation EU/3/21/2512
Active substance: Idursulfase beta
Designated 2021-10-15. Orphan designation status: Positive.
First published: 2022-01-21.

Intended use: Treatment of mucopolysaccharidosis type II (Hunter's syndrome)

Source: https://www.ema.europa.eu/en/medicines/human/orphan-designations/eu-3-21-2512